EMA orphan designation EU/3/00/003
Active substance: alpha-galactosidase A
Designated 2000-08-08. Orphan designation status: Expired.
First published: 2009-11-19. Last updated: 2011-11-18.

Intended use: Treatment of Fabry disease

Source: https://www.ema.europa.eu/en/medicines/human/orphan-designations/eu-3-00-003